EMA orphan designation EU/3/10/789
Active substance: 16-base single-stranded peptide nucleic acid oligonucleotide linked to 7-amino acid peptide
Designated 2010-10-01. Orphan designation status: Positive.
First published: 2010-10-14. Last updated: 2014-04-01.

Intended use: Treatment of medulloblastoma

Source: https://www.ema.europa.eu/en/medicines/human/orphan-designations/eu-3-10-789